EMA orphan designation EU/3/19/2227: Romvimza
Active substance: 2-(isopropylamino)-3-methyl-5-(6-methyl-5-((2-(1-methyl-1H-pyrazol-4-yl)pyridin-4-yl)oxy)pyridin-2-yl)pyrimidin-4(3H)-one
Designated 2019-12-16. Orphan designation status: Positive. EMA product number: EMEA/H/C/006363.
First published: 2020-02-24. Last updated: 2021-08-25.

Intended use: Treatment of tenosynovial giant cell tumour, localised and diffuse type

Source: https://www.ema.europa.eu/en/medicines/human/orphan-designations/eu-3-19-2227